EMA orphan designation EU/3/16/1713
Active substance: 2-(1,5-dimethyl-3-phenyl-1H-pyrrol-2-yl)-N-{4-[4-(5-fluoro-pyrimidin-2-yl)piperazin-1-yl]-phenyl}-2-oxo-acetamide (also known as F901318) (olorofim)
Designated 2016-08-29. Orphan designation status: Positive.
First published: 2016-10-03. Last updated: 2024-04-08.

Intended use: Treatment of scedosporiosis

Source: https://www.ema.europa.eu/en/medicines/human/orphan-designations/eu-3-16-1713